EMA orphan designation EU/3/21/2517: Lunsumio
Active substance: mosunetuzumab
Designated 2021-11-12. Orphan designation status: Positive. EMA product number: EMEA/H/C/005680.
First published: 2022-06-08. Last updated: 2022-06-23.

Intended use: Treatment of follicular lymphoma

Source: https://www.ema.europa.eu/en/medicines/human/orphan-designations/eu-3-21-2517